EMA orphan designation EU/3/14/1416
Active substance: chimeric monoclonal antibody to O-acetyl-GD2 antigen
Designated 2015-01-15. Orphan designation status: Positive.
First published: 2015-03-05. Last updated: 2016-08-15.

Intended use: Treatment of neuroblastoma

Source: https://www.ema.europa.eu/en/medicines/human/orphan-designations/eu-3-14-1416